EMA orphan designation EU/3/24/2972
Active substance: sodium valproate
Designated 2024-08-21. Orphan designation status: Positive.
First published: 2024-09-19.

Intended use: Treatment of pulmonary arterial hypertension

Source: https://www.ema.europa.eu/en/medicines/human/orphan-designations/eu-3-24-2972